EMA orphan designation EU/3/05/329
Active substance: Peptide 144 TGF-beta1-inhibitor (TSLDASIIWAMMQN)
Designated 2005-10-28. Orphan designation status: Positive.
First published: 2007-05-30. Last updated: 2010-06-23.

Intended use: Treatment of localised scleroderma

Source: https://www.ema.europa.eu/en/medicines/human/orphan-designations/eu-3-05-329